EMA orphan designation EU/3/22/2724
Active substance: 16-base single stranded RNA targeting miR-23b linked to oleic acid
Designated 2022-12-09. Orphan designation status: Positive.
First published: 2023-03-13.

Intended use: Treatment of myotonic disorders

Source: https://www.ema.europa.eu/en/medicines/human/orphan-designations/eu-3-22-2724